EMA orphan designation EU/3/11/906
Active substance: Kifunensine
Designated 2011-09-27. Orphan designation status: Withdrawn.
First published: 2011-10-17. Last updated: 2014-06-19.

Intended use: Treatment of beta sarcoglycanopathy

Source: https://www.ema.europa.eu/en/medicines/human/orphan-designations/eu-3-11-906